EMA orphan designation EU/3/13/1110
Active substance: recombinant human heat-shock protein 70
Designated 2013-03-12. Orphan designation status: Withdrawn.
First published: 2013-04-05. Last updated: 2017-08-14.

Intended use: Treatment of Niemann-Pick's disease, type C

Source: https://www.ema.europa.eu/en/medicines/human/orphan-designations/eu-3-13-1110